EMA orphan designation EU/3/12/1050
Active substance: [2-Cyano-3-cyclopropyl-3-hydroxy-N-(3-methyl-4-trifluoromethylphenyl)prop-2-enamide]
Designated 2012-10-10. Orphan designation status: Positive.
First published: 2012-11-16. Last updated: 2012-11-16.

Intended use: Treatment of traumatic spinal cord injury

Source: https://www.ema.europa.eu/en/medicines/human/orphan-designations/eu-3-12-1050